EMA orphan designation EU/3/06/390
Active substance: 4-Amino-(6R,S)-5,6,7,8-tetrahydro-L-biopterin dihydrochloride (ronopterin)
Designated 2006-08-28. Orphan designation status: Positive.
First published: 2009-02-24. Last updated: 2023-08-11.

Intended use: Treatment of moderate and severe traumatic brain injury

Source: https://www.ema.europa.eu/en/medicines/human/orphan-designations/eu-3-06-390